EMA orphan designation EU/3/16/1737
Active substance: (E)-(6-((N-methyl-((3-methylbenzofuran-2-yl)methyl)amino)-3-oxoprop-1-en-1-yl)-2-oxo-3,4-dihydro-1,8-naphthyridin-1(2H)-yl)methyl phosphate, bis ethanolamine salt (afabicin)
Designated 2016-10-14. Orphan designation status: Positive.
First published: 2016-11-15. Last updated: 2021-10-13.

Intended use: Treatment of osteomyelitis

Source: https://www.ema.europa.eu/en/medicines/human/orphan-designations/eu-3-16-1737